EMA orphan designation EU/3/08/561: Luxceptar
Active substance: Donor lymphocyte preparation depleted of functional alloreactive T-cells
Designated 2008-09-05. Orphan designation status: Withdrawn. EMA product number: EMEA/H/C/002397.
First published: 2009-04-24. Last updated: 2022-02-28.

Intended use: Prevention of graft-versus-host disease

Source: https://www.ema.europa.eu/en/medicines/human/orphan-designations/eu-3-08-561